EMA orphan designation EU/3/19/2209
Active substance: anti-neonatal Fc receptor human monoclonal antibody
Designated 2019-10-17. Orphan designation status: Positive.
First published: 2020-01-22. Last updated: 2021-05-05.

Intended use: Prevention of haemolytic disease of the foetus and newborn

Source: https://www.ema.europa.eu/en/medicines/human/orphan-designations/eu-3-19-2209